EMA orphan designation EU/3/11/852
Active substance: human anthrax monoclonal antibody
Designated 2011-04-15. Orphan designation status: Withdrawn.
First published: 2011-04-26. Last updated: 2016-08-11.

Intended use: Treatment of inhalation anthrax disease

Source: https://www.ema.europa.eu/en/medicines/human/orphan-designations/eu-3-11-852